EMA orphan designation EU/3/10/751
Active substance: synthetic double-stranded siRNA oligonucleotide directed against p53 mRNA (teprasiran)
Designated 2010-06-06. Orphan designation status: Withdrawn.
First published: 2010-06-23. Last updated: 2022-02-28.

Intended use: Prevention of delayed graft function after renal transplantation

Source: https://www.ema.europa.eu/en/medicines/human/orphan-designations/eu-3-10-751